EMA orphan designation EU/3/07/493
Active substance: Isofagomine tartrate
Designated 2007-10-23. Orphan designation status: Withdrawn.
First published: 2009-09-21. Last updated: 2019-02-21.

Intended use: Treatment of Gaucher disease

Source: https://www.ema.europa.eu/en/medicines/human/orphan-designations/eu-3-07-493